EMA orphan designation EU/3/20/2287
Active substance: adeno-associated virus serotype 9 containing the human ASPA gene
Designated 2020-06-04. Orphan designation status: Positive.
First published: 2020-08-19. Last updated: 2021-04-06.

Intended use: Treatment of Canavan disease

Source: https://www.ema.europa.eu/en/medicines/human/orphan-designations/eu-3-20-2287